EMA orphan designation EU/3/05/334
Orphan designation status: Positive.
First published: 2007-12-04. Last updated: 2025-03-11.

Intended use: Treatment of X-linked hypohidrotic ectodermal dysplasia (Christ-Siemens-Touraine Syndrome)

Source: https://www.ema.europa.eu/en/medicines/human/orphan-designations/eu-3-05-334